EMA orphan designation EU/3/19/2178
Active substance: sodium benzoate; sodium phenylacetate
Designated 2019-06-28. Orphan designation status: Positive.
First published: 2019-10-14.

Intended use: Treatment of argininosuccinic aciduria

Source: https://www.ema.europa.eu/en/medicines/human/orphan-designations/eu-3-19-2178